EMA orphan designation EU/3/21/2444
Active substance: Humanised IgG2 monoclonal antibody against TNFSF13
Designated 2021-06-21. Orphan designation status: Positive.
First published: 2022-05-11.

Intended use: Treatment of primary IgA nephropathy

Source: https://www.ema.europa.eu/en/medicines/human/orphan-designations/eu-3-21-2444